EMA orphan designation EU/3/25/3118
Active substance: mRNA encoding Cas12HF endonuclease, Single guide RNA against the human HAO1 gene
Designated 2025-08-22. Orphan designation status: Positive.
First published: 2026-04-10.

Intended use: Treatment of primary hyperoxaluria

Source: https://www.ema.europa.eu/en/medicines/human/orphan-designations/eu-3-25-3118